EMA orphan designation EU/3/14/1397
Active substance: amikacin sulfate
Designated 2014-12-16. Orphan designation status: Positive.
First published: 2015-02-26. Last updated: 2015-02-26.

Intended use: Treatment of Pseudomonas aeruginosa lung infection in cystic fibrosis

Source: https://www.ema.europa.eu/en/medicines/human/orphan-designations/eu-3-14-1397